EMA orphan designation EU/3/05/281
Active substance: Interferon gamma
Designated 2005-05-27. Orphan designation status: Withdrawn.
First published: 2007-05-30. Last updated: 2007-05-30.

Intended use: Treatment of idiopathic pulmonary fibrosis

Source: https://www.ema.europa.eu/en/medicines/human/orphan-designations/eu-3-05-281